EMA orphan designation EU/3/10/778
Active substance: cysteamine bitartrate (gastroresistant) (mercaptamine)
Designated 2010-09-20. Orphan designation status: Positive.
First published: 2010-10-18. Last updated: 2013-10-10.

Intended use: Treatment of cystinosis

Source: https://www.ema.europa.eu/en/medicines/human/orphan-designations/eu-3-10-778